EMA orphan designation EU/3/14/1274
Active substance: Paclitaxel-succinate-Arg-Arg-Leu-Ser-Tyr-Ser-Arg-Arg-Arg-Phe
Designated 2014-06-04. Orphan designation status: Positive.
First published: 2014-07-10. Last updated: 2015-05-27.

Intended use: Treatment of glioma

Source: https://www.ema.europa.eu/en/medicines/human/orphan-designations/eu-3-14-1274